EMA orphan designation EU/3/15/1445
Active substance: ulocuplumab
Designated 2015-02-12. Orphan designation status: Withdrawn.
First published: 2015-04-09. Last updated: 2020-01-13.

Intended use: Treatment of acute myeloid leukaemia

Source: https://www.ema.europa.eu/en/medicines/human/orphan-designations/eu-3-15-1445